EMA orphan designation EU/3/14/1246
Active substance: satoreotide trizoxetan
Designated 2014-02-19. Orphan designation status: Positive.
First published: 2014-04-02. Last updated: 2025-07-17.

Intended use: Diagnosis of gastro-entero-pancreatic neuroendocrine tumours

Source: https://www.ema.europa.eu/en/medicines/human/orphan-designations/eu-3-14-1246